EMA orphan designation EU/3/25/3159
Active substance: orvepitant maleate
Designated 2025-11-21. Orphan designation status: Withdrawn.
First published: 2026-04-10. Last updated: 2026-05-18.

Intended use: Treatment of idiopathic pulmonary fibrosis

Source: https://www.ema.europa.eu/en/medicines/human/orphan-designations/eu-3-25-3159